EMA orphan designation EU/3/17/1938
Active substance: antisense oligonucleotide targeting exon 73 in the COL7A1 gene
Designated 2017-10-12. Orphan designation status: Withdrawn.
First published: 2018-01-15. Last updated: 2024-04-11.

Intended use: Treatment of epidermolysis bullosa

Source: https://www.ema.europa.eu/en/medicines/human/orphan-designations/eu-3-17-1938